EMA orphan designation EU/3/09/698
Active substance: pegylated carboxyhaemoglobin
Designated 2009-11-26. Orphan designation status: Positive.
First published: 2009-12-10. Last updated: 2021-10-13.

Intended use: Treatment of sickle cell disease

Source: https://www.ema.europa.eu/en/medicines/human/orphan-designations/eu-3-09-698